EMA orphan designation EU/3/22/2708
Active substance: acetylleucine
Designated 2022-10-11. Orphan designation status: Positive.
First published: 2023-01-24.

Intended use: Treatment of GM1 gangliosidosis

Source: https://www.ema.europa.eu/en/medicines/human/orphan-designations/eu-3-22-2708